EMA orphan designation EU/3/17/1841
Active substance: propranolol hydrochloride
Designated 2017-02-27. Orphan designation status: Positive.
First published: 2017-03-31. Last updated: 2017-03-31.

Intended use: Treatment of von Hippel-Lindau disease

Source: https://www.ema.europa.eu/en/medicines/human/orphan-designations/eu-3-17-1841